EMA orphan designation EU/3/12/1069
Active substance: adeno-associated viral vector encoding an inducible short hairpin RNA targeting claudin-5
Designated 2012-11-08. Orphan designation status: Withdrawn.
First published: 2012-12-13. Last updated: 2016-10-21.

Intended use: Treatment of retinitis pigmentosa

Source: https://www.ema.europa.eu/en/medicines/human/orphan-designations/eu-3-12-1069